EMA orphan designation EU/3/08/582
Active substance: recombinant human heparan-N-sulfatase
Designated 2008-11-07. Orphan designation status: Withdrawn.
First published: 2009-06-29. Last updated: 2021-09-02.

Intended use: Treatment of mucopolysaccharidosis, type IIIA (Sanfilippo A syndrome)

Source: https://www.ema.europa.eu/en/medicines/human/orphan-designations/eu-3-08-582